EMA orphan designation EU/3/09/617
Active substance: 2,2-Dimethylbutyric acid, sodium salt
Designated 2009-02-27. Orphan designation status: Positive.
First published: 2009-06-29. Last updated: 2015-03-20.

Intended use: Treatment of beta thalassaemia intermedia and major

Source: https://www.ema.europa.eu/en/medicines/human/orphan-designations/eu-3-09-617